EMA orphan designation EU/3/15/1604
Active substance: two allogeneic irradiated pancreatic tumour cell lines
Designated 2016-01-11. Orphan designation status: Withdrawn.
First published: 2016-02-05. Last updated: 2020-01-13.

Intended use: Treatment of pancreatic cancer

Source: https://www.ema.europa.eu/en/medicines/human/orphan-designations/eu-3-15-1604